EMA orphan designation EU/3/17/1867
Active substance: modified messenger ribonucleic acid encoding human ornithine transcarbamylase enzyme encapsulated into lipid nanoparticles
Designated 2017-04-20. Orphan designation status: Positive.
First published: 2017-05-15. Last updated: 2017-05-15.

Intended use: Treatment of ornithine transcarbamylase deficiency

Source: https://www.ema.europa.eu/en/medicines/human/orphan-designations/eu-3-17-1867